EMA orphan designation EU/3/21/2434: Ojemda
Active substance: 6-amino-5-chloro-N-((1R)-1-(5-(((5-chloro-4-(trifluoromethyl)-2-pyridinyl)amino)carbonyl)-2-thiazolyl)ethyl)-4-pyrimidinecarboxamide
Designated 2021-05-20. Orphan designation status: Positive. EMA product number: EMEA/H/C/006140.
First published: 2022-03-18. Last updated: 2024-10-31.

Intended use: Treatment of glioma

Source: https://www.ema.europa.eu/en/medicines/human/orphan-designations/eu-3-21-2434